EMA orphan designation EU/3/20/2305
Active substance: imetelstat sodium
Designated 2020-07-27. Orphan designation status: Positive.
First published: 2020-11-19. Last updated: 2025-04-09.

Intended use: Treatment of myelodysplastic syndromes

Source: https://www.ema.europa.eu/en/medicines/human/orphan-designations/eu-3-20-2305